EMA orphan designation EU/3/01/067
Active substance: thalidomide
Designated 2001-11-20. Orphan designation status: Withdrawn.
First published: 2008-07-29. Last updated: 2018-07-18.

Intended use: Treatment of multiple myeloma

Source: https://www.ema.europa.eu/en/medicines/human/orphan-designations/eu-3-01-067